EMA orphan designation EU/3/01/078
Active substance: Iduronate-2-sulfatase
Designated 2001-12-11. Orphan designation status: Expired.
First published: 2010-10-18. Last updated: 2017-02-08.

Intended use: Treatment of mucopolysaccharidosis type II (Hunter's syndrome)

Source: https://www.ema.europa.eu/en/medicines/human/orphan-designations/eu-3-01-078